EMA orphan designation EU/3/07/435
Active substance: recombinant human C1-inhibitor
Designated 2007-02-20. Orphan designation status: Withdrawn.
First published: 2009-09-23. Last updated: 2009-09-23.

Intended use: Prevention of delayed graft function after solid organ transplantation

Source: https://www.ema.europa.eu/en/medicines/human/orphan-designations/eu-3-07-435